EMA orphan designation EU/3/23/2865
Active substance: selumetinib
Designated 2023-12-13. Orphan designation status: Positive.
First published: 2024-02-01.

Intended use: Treatment of glioma

Source: https://www.ema.europa.eu/en/medicines/human/orphan-designations/eu-3-23-2865